EMA orphan designation EU/3/01/018
Active substance: recombinant human acid alpha-glucosidase
Designated 2001-02-15. Orphan designation status: Expired.
First published: 2009-06-15. Last updated: 2016-08-10.

Intended use: Treatment of glycogen storage disease type II (Pompe's disease)

Source: https://www.ema.europa.eu/en/medicines/human/orphan-designations/eu-3-01-018